EMA orphan designation EU/3/24/2903
Active substance: Mibavademab
Designated 2024-03-21. Orphan designation status: Positive.
First published: 2024-07-23.

Intended use: Treatment of Berardinelli-Seip syndrome (congenital generalised lipodystrophy)

Source: https://www.ema.europa.eu/en/medicines/human/orphan-designations/eu-3-24-2903